EMA orphan designation EU/3/17/1854
Active substance: autologous adipose tissue-derived mesenchymal stem cells
Designated 2017-03-20. Orphan designation status: Withdrawn.
First published: 2017-05-05. Last updated: 2026-05-18.

Intended use: Treatment of thromboangiitis obliterans (Buerger's disease)

Source: https://www.ema.europa.eu/en/medicines/human/orphan-designations/eu-3-17-1854